EMA orphan designation EU/3/11/940
Active substance: chimeric locked nucleic acid-deoxynucleoside phosphorothioate-linked oligonucleotide directed against microRNA-451
Designated 2012-01-11. Orphan designation status: Withdrawn.
First published: 2012-02-01. Last updated: 2017-05-17.

Intended use: Treatment of polycythaemia vera

Source: https://www.ema.europa.eu/en/medicines/human/orphan-designations/eu-3-11-940